EMA orphan designation EU/3/25/3070
Active substance: empasiprubart
Designated 2025-06-20. Orphan designation status: Positive.
First published: 2025-07-25.

Intended use: Treatment of multifocal motor neuropathy

Source: https://www.ema.europa.eu/en/medicines/human/orphan-designations/eu-3-25-3070